EMA orphan designation EU/3/01/046
Active substance: thalidomide
Designated 2001-07-09. Orphan designation status: Withdrawn.
First published: 2009-10-15. Last updated: 2009-10-15.

Intended use: Treatment of graft-versus-host disease

Source: https://www.ema.europa.eu/en/medicines/human/orphan-designations/eu-3-01-046